EMA orphan designation EU/3/10/794: Inrebic
Active substance: N-tert-butyl-3-[(5-methyl-2-{[4-(2-pyrrolidin-1-ylethoxy)phenyl]amino}pyrimidin-4-yl)amino] benzenesulfonamide dihydrochloride monohydrate (fedratinib)
Designated 2010-10-01. Orphan designation status: Positive. EMA product number: EMEA/H/C/005026.
First published: 2010-10-14. Last updated: 2021-09-17.

Intended use: Treatment of primary myelofibrosis

Source: https://www.ema.europa.eu/en/medicines/human/orphan-designations/eu-3-10-794